EMA orphan designation EU/3/23/2879
Active substance: Ersodetug
Designated 2024-01-12. Orphan designation status: Positive.
First published: 2024-04-11.

Intended use: Treatment of insulinoma

Source: https://www.ema.europa.eu/en/medicines/human/orphan-designations/eu-3-23-2879